EMA orphan designation EU/3/22/2652
Active substance: 3-(1,3-benzodioxol-5-yl)-5-(3-bromophenyl)-1H-pyrazole
Designated 2022-07-18. Orphan designation status: Positive.
First published: 2023-01-23.

Intended use: Treatment of multiple system atrophy

Source: https://www.ema.europa.eu/en/medicines/human/orphan-designations/eu-3-22-2652